EMA orphan designation EU/3/09/704
Active substance: givinostat
Designated 2010-01-28. Orphan designation status: Withdrawn.
First published: 2010-02-25. Last updated: 2013-06-25.

Intended use: Treatment of systemic-onset juvenile idiopathic arthritis

Source: https://www.ema.europa.eu/en/medicines/human/orphan-designations/eu-3-09-704